EMA orphan designation EU/3/14/1259: Arikayce liposomal
Active substance: amikacin sulfate
Designated 2014-04-08. Orphan designation status: Positive. EMA product number: EMEA/H/C/005264.
First published: 2014-05-07. Last updated: 2020-11-03.

Intended use: Treatment of nontuberculous mycobacterial lung disease

Source: https://www.ema.europa.eu/en/medicines/human/orphan-designations/eu-3-14-1259